EMA orphan designation EU/3/25/3048
Active substance: Adeno-associated viral vector serotype LK03 containing the human CFI gene
Designated 2025-04-16. Orphan designation status: Positive.
First published: 2025-06-10.

Intended use: Treatment of primary IgA nephropathy

Source: https://www.ema.europa.eu/en/medicines/human/orphan-designations/eu-3-25-3048